EMA orphan designation EU/3/01/044
Active substance: human alpha1-proteinase inhibitor
Designated 2001-07-09. Orphan designation status: Positive.
First published: 2009-02-24. Last updated: 2018-03-28.

Intended use: Treatment of emphysema secondary to congenital alpha-1 antitrypsin deficiency

Source: https://www.ema.europa.eu/en/medicines/human/orphan-designations/eu-3-01-044